EMA orphan designation EU/3/14/1352
Active substance: raxibacumab
Designated 2014-10-15. Orphan designation status: Positive.
First published: 2014-11-12.

Intended use: Treatment of inhalation anthrax disease

Source: https://www.ema.europa.eu/en/medicines/human/orphan-designations/eu-3-14-1352